EMA orphan designation EU/3/26/3202
Active substance: 1-{[2-(3,6-Diazabicyclo[3.1.1]heptan-3-yl)-7-(1,3-thiazol-2-yl)-1,3-benzoxazol-4-yl]oxy}-1,1-difluoro-2-methylpropan-2-ol
Orphan designation status: Positive.
First published: 2026-04-14.

Intended use: Treatment of palmoplantar pustulosis

Source: https://www.ema.europa.eu/en/medicines/human/orphan-designations/eu-3-26-3202